EMA orphan designation EU/3/17/1923: Daurismo
Active substance: Glasdegib maleate
Designated 2017-10-16. Orphan designation status: Positive. EMA product number: EMEA/H/C/004878.
First published: 2018-01-09. Last updated: 2018-01-09.

Intended use: Treatment of acute myeloid leukaemia

Source: https://www.ema.europa.eu/en/medicines/human/orphan-designations/eu-3-17-1923